EMA orphan designation EU/3/14/1323
Active substance: [5-Amino-1-(4-fluoro-phenyl)-1H-pyrazol-4-yl]-[3-(2,3-dihydroxy-propoxy)-phenyl]-methanone
Designated 2014-08-22. Orphan designation status: Positive.
First published: 2014-10-02. Last updated: 2014-10-02.

Intended use: Treatment of pancreatic cancer

Source: https://www.ema.europa.eu/en/medicines/human/orphan-designations/eu-3-14-1323